EMA orphan designation EU/3/22/2648
Active substance: thiostrepton
Designated 2022-07-18. Orphan designation status: Positive.
First published: 2023-01-23.

Intended use: Treatment of malignant mesothelioma

Source: https://www.ema.europa.eu/en/medicines/human/orphan-designations/eu-3-22-2648